EMA orphan designation EU/3/16/1675
Active substance: citric acid monohydrate
Designated 2016-06-27. Orphan designation status: Positive.
First published: 2016-07-26. Last updated: 2023-06-05.

Intended use: Treatment of acute liver failure

Source: https://www.ema.europa.eu/en/medicines/human/orphan-designations/eu-3-16-1675